EMA orphan designation EU/3/25/3059
Active substance: 1-{4-[2-(5-ethoxymethyl-2-methyl-phenylamino)-oxazol-5-yl]-phenyl}-imidazolidin-2-one
Designated 2025-05-22. Orphan designation status: Positive.
First published: 2025-06-30.

Intended use: Treatment of acute myeloid leukaemia

Source: https://www.ema.europa.eu/en/medicines/human/orphan-designations/eu-3-25-3059